EMA orphan designation EU/3/12/964
Active substance: oleylphosphocholine
Designated 2012-04-23. Orphan designation status: Positive.
First published: 2012-05-03. Last updated: 2020-07-10.

Intended use: Treatment of leishmaniasis

Source: https://www.ema.europa.eu/en/medicines/human/orphan-designations/eu-3-12-964